EMA orphan designation EU/3/21/2469
Designated 2021-07-19. Orphan designation status: Positive.
First published: 2021-11-12.

Intended use: Treatment of chronic myeloid leukaemia

Source: https://www.ema.europa.eu/en/medicines/human/orphan-designations/eu-3-21-2469